EMA orphan designation EU/3/16/1751
Active substance: Fenretinide
Designated 2016-10-14. Orphan designation status: Withdrawn.
First published: 2016-11-15. Last updated: 2022-01-19.

Intended use: Treatment of peripheral T-cell lymphoma

Source: https://www.ema.europa.eu/en/medicines/human/orphan-designations/eu-3-16-1751